EMA orphan designation EU/3/12/1005
Active substance: talarozole
Designated 2012-07-04. Orphan designation status: Withdrawn.
First published: 2012-07-24. Last updated: 2016-01-19.

Intended use: Treatment of autosomal recessive congenital ichthyosis

Source: https://www.ema.europa.eu/en/medicines/human/orphan-designations/eu-3-12-1005